EMA orphan designation EU/3/15/1576
Active substance: xevinapant
Designated 2015-11-11. Orphan designation status: Withdrawn.
First published: 2016-01-06. Last updated: 2025-02-20.

Intended use: Treatment of ovarian cancer

Source: https://www.ema.europa.eu/en/medicines/human/orphan-designations/eu-3-15-1576